EMA orphan designation EU/3/18/2040
Active substance: recombinant human placental growth factor
Designated 2018-06-27. Orphan designation status: Positive.
First published: 2018-08-22. Last updated: 2018-08-22.

Intended use: Treatment of pre-eclampsia

Source: https://www.ema.europa.eu/en/medicines/human/orphan-designations/eu-3-18-2040